EMA orphan designation EU/3/15/1566
Active substance: autologous human peripheral blood Vdelta1+ T lymphocytes activated in vitro by cytokine and monoclonal antibody treatment
Designated 2015-10-09. Orphan designation status: Positive.
First published: 2015-11-16. Last updated: 2015-11-16.

Intended use: Treatment of chronic lymphocytic leukaemia / small lymphocytic lymphoma

Source: https://www.ema.europa.eu/en/medicines/human/orphan-designations/eu-3-15-1566